EMA orphan designation EU/3/13/1139
Active substance: sodium chlorite
Designated 2013-06-19. Orphan designation status: Positive.
First published: 2013-07-11. Last updated: 2018-01-03.

Intended use: Treatment of amyotrophic lateral sclerosis

Source: https://www.ema.europa.eu/en/medicines/human/orphan-designations/eu-3-13-1139